EMA orphan designation EU/3/20/2275
Active substance: Glucagon analogue linked to a human immunoglobulin Fc fragment
Designated 2020-04-22. Orphan designation status: Positive.
First published: 2020-08-13.

Intended use: Treatment of insulin autoimmune syndrome

Source: https://www.ema.europa.eu/en/medicines/human/orphan-designations/eu-3-20-2275